EMA orphan designation EU/3/10/735: Ravicti
Active substance: Glyceryl tri-(4-phenylbutyrate)
Designated 2010-06-10. Orphan designation status: Positive. EMA product number: EMEA/H/C/003822.
First published: 2010-06-28. Last updated: 2022-11-18.

Intended use: Treatment of citrullinaemia type 1

Source: https://www.ema.europa.eu/en/medicines/human/orphan-designations/eu-3-10-735